EMA orphan designation EU/3/22/2700
Active substance: Adeno-associated viral vector serotype rh.10 encoding the CLN2 gene
Designated 2022-10-11. Orphan designation status: Positive.
First published: 2023-01-24. Last updated: 2024-10-15.

Intended use: Treatment of neuronal ceroid lipofuscinosis

Source: https://www.ema.europa.eu/en/medicines/human/orphan-designations/eu-3-22-2700